EMA orphan designation EU/3/09/701
Active substance: 2-iminobiotin
Designated 2010-01-28. Orphan designation status: Positive.
First published: 2010-02-25. Last updated: 2015-05-13.

Intended use: Treatment of perinatal asphyxia

Source: https://www.ema.europa.eu/en/medicines/human/orphan-designations/eu-3-09-701